EMA orphan designation EU/3/21/2408
Active substance: Adeno-associated virus serotype 5 containing the human NR2E3 gene
Designated 2021-02-19. Orphan designation status: Positive.
First published: 2022-02-23.

Intended use: Treatment of retinitis pigmentosa

Source: https://www.ema.europa.eu/en/medicines/human/orphan-designations/eu-3-21-2408